EMA orphan designation EU/3/09/651
Active substance: ciclosporin
Designated 2009-07-24. Orphan designation status: Positive.
First published: 2009-09-11. Last updated: 2009-09-11.

Intended use: Treatment of atopic keratoconjunctivitis

Source: https://www.ema.europa.eu/en/medicines/human/orphan-designations/eu-3-09-651